EMA orphan designation EU/3/10/802
Active substance: 2-(2-chlorophenyl)-4-[3-(dimethylamino)phenyl]-5-methyl-1H-pyrazolo[4,3-C]pyridine-3,6(2H,5H)-dione
Designated 2010-11-26. Orphan designation status: Positive.
First published: 2010-12-15. Last updated: 2022-08-30.

Intended use: Treatment of idiopathic pulmonary fibrosis

Source: https://www.ema.europa.eu/en/medicines/human/orphan-designations/eu-3-10-802